EMA orphan designation EU/3/24/3014
Active substance: arsenic trioxide
Designated 2024-12-13. Orphan designation status: Positive.
First published: 2025-01-14. Last updated: 2025-02-24.

Intended use: Treatment of acute promyelocytic leukaemia

Source: https://www.ema.europa.eu/en/medicines/human/orphan-designations/eu-3-24-3014